EMA orphan designation EU/3/13/1205
Active substance: human monoclonal antibody against human interleukin 13
Designated 2013-11-13. Orphan designation status: Withdrawn.
First published: 2013-12-02. Last updated: 2022-08-30.

Intended use: Treatment of eosinophilic oesophagitis

Source: https://www.ema.europa.eu/en/medicines/human/orphan-designations/eu-3-13-1205